EMA orphan designation EU/3/11/926
Active substance: recombinant human minibody against complement component C5
Designated 2011-10-27. Orphan designation status: Withdrawn.
First published: 2011-11-23. Last updated: 2019-04-12.

Intended use: Treatment of primary membranoproliferative glomerulonephritis

Source: https://www.ema.europa.eu/en/medicines/human/orphan-designations/eu-3-11-926